EMA orphan designation EU/3/23/2764
Active substance: guanabenz acetate
Designated 2023-03-20. Orphan designation status: Positive.
First published: 2024-01-31.

Intended use: Treatment of vanishing white matter disease

Source: https://www.ema.europa.eu/en/medicines/human/orphan-designations/eu-3-23-2764